EMA orphan designation EU/3/14/1290
Active substance: adeno-associated viral vector serotype 2 containing the human REP1 gene
Designated 2014-07-04. Orphan designation status: Positive.
First published: 2014-07-15. Last updated: 2019-04-12.

Intended use: Treatment of choroideraemia

Source: https://www.ema.europa.eu/en/medicines/human/orphan-designations/eu-3-14-1290